EMA orphan designation EU/3/08/542
Active substance: ribonucleotide reductase R2 specific phosphorothioate oligonucleotide
Designated 2008-05-08. Orphan designation status: Positive.
First published: 2008-07-29. Last updated: 2022-03-18.

Intended use: Treatment of acute myeloid leukaemia

Source: https://www.ema.europa.eu/en/medicines/human/orphan-designations/eu-3-08-542